EMA orphan designation EU/3/13/1167
Active substance: heterologous human adult liver-derived progenitor cells
Designated 2013-07-17. Orphan designation status: Positive.
First published: 2013-08-22. Last updated: 2024-10-28.

Intended use: Treatment of ornithine-translocase deficiency

Source: https://www.ema.europa.eu/en/medicines/human/orphan-designations/eu-3-13-1167